EMA orphan designation EU/3/21/2427
Active substance: begelomab
Designated 2021-05-20. Orphan designation status: Positive.
First published: 2022-03-16.

Intended use: Treatment of dermatomyositis

Source: https://www.ema.europa.eu/en/medicines/human/orphan-designations/eu-3-21-2427